EMA orphan designation EU/3/13/1207
Active substance: trebananib
Designated 2013-11-13. Orphan designation status: Positive.
First published: 2013-12-02. Last updated: 2013-12-02.

Intended use: Treatment of ovarian cancer

Source: https://www.ema.europa.eu/en/medicines/human/orphan-designations/eu-3-13-1207